EMA orphan designation EU/3/25/3153
Active substance: vopimetostat
Designated 2025-11-21. Orphan designation status: Positive.
First published: 2026-04-10.

Intended use: Treatment of pancreatic cancer

Source: https://www.ema.europa.eu/en/medicines/human/orphan-designations/eu-3-25-3153